EMA orphan designation EU/3/09/647
Active substance: (S)-3'-(OH)-desazadesferrithiocin-polyether, magnesium salt (deferitazole)
Designated 2009-07-24. Orphan designation status: Withdrawn.
First published: 2009-09-11. Last updated: 2018-03-26.

Intended use: Treatment of chronic iron overload requiring chelation therapy

Source: https://www.ema.europa.eu/en/medicines/human/orphan-designations/eu-3-09-647